EMA orphan designation EU/3/25/3193
Active substance: spironolactone
Designated 2026-01-09. Orphan designation status: Positive.
First published: 2026-04-10.

Intended use: Treatment of central serous chorioretinopathy

Source: https://www.ema.europa.eu/en/medicines/human/orphan-designations/eu-3-25-3193